EMA orphan designation EU/3/22/2661
Active substance: 2,4,6,7,8,9-hexahydro-4-((2-methylphenyl)methyl)-7-(phenylmethyl)imidazo(1,2-a)pyrido(3,4-e)pyrimidin-5(1H)-one
Designated 2022-07-18. Orphan designation status: Positive.
First published: 2023-01-25.

Intended use: Treatment of glioma

Source: https://www.ema.europa.eu/en/medicines/human/orphan-designations/eu-3-22-2661